EMA orphan designation EU/3/18/2003
Active substance: ribavirin
Designated 2018-02-21. Orphan designation status: Positive.
First published: 2018-05-15. Last updated: 2021-04-12.

Intended use: Treatment of Lassa fever

Source: https://www.ema.europa.eu/en/medicines/human/orphan-designations/eu-3-18-2003